EMA orphan designation EU/3/09/706
Active substance: Lithium citrate tetrahydrate
Designated 2010-01-28. Orphan designation status: Positive.
First published: 2010-02-25. Last updated: 2010-02-25.

Intended use: Treatment of Huntington’s disease

Source: https://www.ema.europa.eu/en/medicines/human/orphan-designations/eu-3-09-706